EMA orphan designation EU/3/16/1766
Active substance: venetoclax
Designated 2016-10-14. Orphan designation status: Withdrawn.
First published: 2016-11-15. Last updated: 2025-03-11.

Intended use: Treatment of diffuse large B-cell lymphoma

Source: https://www.ema.europa.eu/en/medicines/human/orphan-designations/eu-3-16-1766